EMA orphan designation EU/3/20/2297
Active substance: adeno-associated virus serotype HSC15 expressing human arylsulfatase A gene
Designated 2020-06-26. Orphan designation status: Withdrawn.
First published: 2020-09-24. Last updated: 2023-07-21.

Intended use: treatment of metachromatic leukodystrophy

Source: https://www.ema.europa.eu/en/medicines/human/orphan-designations/eu-3-20-2297